EMA orphan designation EU/3/02/085
Active substance: carmustine
Designated 2002-03-05. Orphan designation status: Withdrawn.
First published: 2003-01-23. Last updated: 2013-10-17.

Intended use: Treatment of glioma

Source: https://www.ema.europa.eu/en/medicines/human/orphan-designations/eu-3-02-085